EMA orphan designation EU/3/18/2065
Active substance: obiltoxaximab
Designated 2018-08-24. Orphan designation status: Withdrawn.
First published: 2018-12-19. Last updated: 2020-11-27.

Intended use: Treatment of anthrax

Source: https://www.ema.europa.eu/en/medicines/human/orphan-designations/eu-3-18-2065